EMA orphan designation EU/3/16/1815
Active substance: fluticasone propionate
Designated 2017-01-12. Orphan designation status: Positive.
First published: 2017-07-14. Last updated: 2022-03-01.

Intended use: Treatment of eosinophilic oesophagitis

Source: https://www.ema.europa.eu/en/medicines/human/orphan-designations/eu-3-16-1815